EMA orphan designation EU/3/09/718
Active substance: Fingolimod
Designated 2010-02-02. Orphan designation status: Withdrawn.
First published: 2010-03-04. Last updated: 2017-02-07.

Intended use: Treatment of chronic inflammatory demyelinating polyneuropathy

Source: https://www.ema.europa.eu/en/medicines/human/orphan-designations/eu-3-09-718